EMA orphan designation EU/3/21/2414
Active substance: lepunafusp alfa
Designated 2021-03-26. Orphan designation status: Positive.
First published: 2022-01-19. Last updated: 2025-07-17.

Intended use: Treatment of mucopolysaccharidosis type I

Source: https://www.ema.europa.eu/en/medicines/human/orphan-designations/eu-3-21-2414